EMA orphan designation EU/3/15/1594
Active substance: Live attenuated Listeria monocytogenes delta actA/delta inlB strain expressing human mesothelin
Designated 2015-12-14. Orphan designation status: Withdrawn.
First published: 2016-02-03. Last updated: 2020-01-13.

Intended use: Treatment of malignant mesothelioma

Source: https://www.ema.europa.eu/en/medicines/human/orphan-designations/eu-3-15-1594